EMA orphan designation EU/3/23/2782
Active substance: aspacytarabine
Designated 2023-05-22. Orphan designation status: Positive.
First published: 2024-02-02.

Intended use: Treatment of myelodysplastic syndromes

Source: https://www.ema.europa.eu/en/medicines/human/orphan-designations/eu-3-23-2782